EMA orphan designation EU/3/25/3180
Active substance: aminolevulinic acid hydrochloride
Designated 2026-01-09. Orphan designation status: Positive.
First published: 2026-04-10.

Intended use: Diagnosis of ovarian cancer

Source: https://www.ema.europa.eu/en/medicines/human/orphan-designations/eu-3-25-3180